EMA orphan designation EU/3/14/1236
Active substance: Diacerein
Designated 2014-02-20. Orphan designation status: Positive.
First published: 2014-03-31. Last updated: 2024-04-11.

Intended use: Treatment of epidermolysis bullosa

Source: https://www.ema.europa.eu/en/medicines/human/orphan-designations/eu-3-14-1236